EMA orphan designation EU/3/20/2346
Active substance: adeno-associated virus serotype hu68 containing the human GLB1 gene
Designated 2020-10-19. Orphan designation status: Positive.
First published: 2021-02-16.

Intended use: Treatment of GM1 gangliosidosis

Source: https://www.ema.europa.eu/en/medicines/human/orphan-designations/eu-3-20-2346